EMA orphan designation EU/3/06/367
Active substance: Parathyroid hormone (1-34) transglutaminase fusion protein fibrin matrix complex
Designated 2006-04-11. Orphan designation status: Positive.
First published: 2009-04-24. Last updated: 2009-04-24.

Intended use: Treatment of solitary bone cysts

Source: https://www.ema.europa.eu/en/medicines/human/orphan-designations/eu-3-06-367